EMA orphan designation EU/3/18/2097
Active substance: Larotrectinib
Designated 2018-11-19. Orphan designation status: Withdrawn.
First published: 2019-02-19. Last updated: 2019-10-24.

Intended use: Treatment of glioma

Source: https://www.ema.europa.eu/en/medicines/human/orphan-designations/eu-3-18-2097